EMA orphan designation EU/3/25/3097
Active substance: humanised IgG1 kappa monoclonal antibody against tyrosine-protein phosphatase non-receptor type substrate 1, signal-regulatory protein beta-1 and signal-regulatory protein gamma
Designated 2025-07-18. Orphan designation status: Positive.
First published: 2025-09-04.

Intended use: Treatment of haemophagocytic lymphohistiocytosis

Source: https://www.ema.europa.eu/en/medicines/human/orphan-designations/eu-3-25-3097